EMA orphan designation EU/3/19/2223
Active substance: Exendin (9-39)
Designated 2019-11-13. Orphan designation status: Positive.
First published: 2020-02-13.

Intended use: Treatment of congenital hyperinsulinism

Source: https://www.ema.europa.eu/en/medicines/human/orphan-designations/eu-3-19-2223